EMA orphan designation EU/3/13/1109
Active substance: 4-[2-(6-Methylpyridin-2-yl)-5,6-dihydro-4H-pyrrolo[1,2-b]pyrazol-3-yl]-quinoline-6-carboxamide monohydrate
Designated 2013-03-12. Orphan designation status: Withdrawn.
First published: 2013-04-05. Last updated: 2019-06-17.

Intended use: Treatment of hepatocellular carcinoma

Source: https://www.ema.europa.eu/en/medicines/human/orphan-designations/eu-3-13-1109